EMA orphan designation EU/3/07/496: Loulla
Active substance: Mercaptopurine
Designated 2007-10-22. Orphan designation status: Positive. EMA product number: EMEA/H/C/002501.
First published: 2008-07-02. Last updated: 2014-01-22.

Intended use: Treatment of acute lymphoblastic leukaemia

Source: https://www.ema.europa.eu/en/medicines/human/orphan-designations/eu-3-07-496